EMA orphan designation EU/3/16/1797
Active substance: arsenic trioxide
Designated 2016-12-12. Orphan designation status: Positive.
First published: 2017-01-10. Last updated: 2018-07-16.

Intended use: Treatment of graft-versus-host disease

Source: https://www.ema.europa.eu/en/medicines/human/orphan-designations/eu-3-16-1797